EMA orphan designation EU/3/14/1300: Reblozyl
Active substance: recombinant fusion protein consisting of a modified form of the extracellular domain of human activin receptor IIB linked to the human IgG1 Fc domain (luspatercept)
Designated 2014-07-29. Orphan designation status: Positive. EMA product number: EMEA/H/C/004444.
First published: 2014-09-01. Last updated: 2023-03-21.

Intended use: Treatment of beta thalassaemia intermedia and major

Source: https://www.ema.europa.eu/en/medicines/human/orphan-designations/eu-3-14-1300